EMA orphan designation EU/3/14/1304: Soliris
Active substance: Eculizumab
Designated 2014-07-29. Orphan designation status: Positive. EMA product number: EMEA/H/C/000791.
First published: 2014-09-01. Last updated: 2019-11-20.

Intended use: Treatment myasthenia gravis

Source: https://www.ema.europa.eu/en/medicines/human/orphan-designations/eu-3-14-1304